EMA orphan designation EU/3/13/1194
Active substance: naproxcinod
Designated 2013-10-07. Orphan designation status: Withdrawn.
First published: 2013-10-31. Last updated: 2018-01-23.

Intended use: Treatment of Duchenne muscular dystrophy

Source: https://www.ema.europa.eu/en/medicines/human/orphan-designations/eu-3-13-1194